EMA orphan designation EU/3/16/1802: Tibsovo; Tidhesco
Active substance: Ivosidenib
Designated 2016-12-12. Orphan designation status: Positive. EMA product number: EMEA/H/C/005936; EMEA/H/C/006174.
First published: 2017-01-09. Last updated: 2023-05-12.

Intended use: Treatment of acute myeloid leukaemia

Source: https://www.ema.europa.eu/en/medicines/human/orphan-designations/eu-3-16-1802